EMA orphan designation EU/3/19/2158
Active substance: (S)-3-((3-(1-((6-(3,4-dimethoxyphenyl)pryazin-2-yl)amino)ethyl)phenyl)carbamoyl)-5-methylpridin-1-ium (seralutinib)
Designated 2019-05-29. Orphan designation status: Positive.
First published: 2019-08-09. Last updated: 2022-09-30.

Intended use: Treatment of pulmonary arterial hypertension

Source: https://www.ema.europa.eu/en/medicines/human/orphan-designations/eu-3-19-2158